EMA orphan designation EU/3/15/1546
Active substance: allogeneic umbilical cord blood cells treated ex vivo with 16,16-dimethyl prostaglandin E2
Designated 2015-08-10. Orphan designation status: Withdrawn.
First published: 2015-10-02. Last updated: 2017-02-07.

Intended use: Treatment of acute lymphoblastic leukaemia

Source: https://www.ema.europa.eu/en/medicines/human/orphan-designations/eu-3-15-1546